EMA orphan designation EU/3/14/1373: Vynpenta (previously AvacopanChemoCentryx); Tavneos
Designated 2014-11-19. Orphan designation status: Positive. EMA product number: EMEA/H/C/004487; EMEA/H/C/005523.
First published: 2015-01-15. Last updated: 2022-07-04.

Intended use: Treatment of granulomatosis with polyangiitis

Source: https://www.ema.europa.eu/en/medicines/human/orphan-designations/eu-3-14-1373